EMA orphan designation EU/3/16/1679
Active substance: eflornithine
Designated 2016-06-27. Orphan designation status: Positive.
First published: 2016-07-26. Last updated: 2016-07-26.

Intended use: Treatment of glioma

Source: https://www.ema.europa.eu/en/medicines/human/orphan-designations/eu-3-16-1679